EMA orphan designation EU/3/17/1845
Active substance: thalidomide
Designated 2017-02-27. Orphan designation status: Positive.
First published: 2017-03-31. Last updated: 2017-03-31.

Intended use: Treatment of hereditary haemorrhagic telangiectasia

Source: https://www.ema.europa.eu/en/medicines/human/orphan-designations/eu-3-17-1845